EMA orphan designation EU/3/07/453: Alprolix
Active substance: recombinant fusion protein consisting of human coagulation factor IX attached to the Fc domain of human IgG1
Designated 2007-06-08. Orphan designation status: Positive. EMA product number: EMEA/H/C/004142.
First published: 2008-07-29. Last updated: 2016-05-30.

Intended use: Treatment of haemophilia B (congenital factor IX deficiency)

Source: https://www.ema.europa.eu/en/medicines/human/orphan-designations/eu-3-07-453